EMA orphan designation EU/3/01/035
Active substance: levodopa; carbidopa
Designated 2001-05-10. Orphan designation status: Withdrawn.
First published: 2009-03-03. Last updated: 2016-01-19.

Intended use: Treatment of advanced idiopathic Parkinson's disease with severe motor fluctuations

Source: https://www.ema.europa.eu/en/medicines/human/orphan-designations/eu-3-01-035